EMA orphan designation EU/3/06/354
Active substance: oxalobacter formigenes strain HC-1
Designated 2006-02-17. Orphan designation status: Positive.
First published: 2009-05-15. Last updated: 2021-02-02.

Intended use: Treatment of primary hyperoxaluria

Source: https://www.ema.europa.eu/en/medicines/human/orphan-designations/eu-3-06-354